EMA orphan designation EU/3/14/1409
Active substance: pegylated recombinant arginine deiminase (pegargiminase)
Designated 2015-01-15. Orphan designation status: Positive.
First published: 2015-03-05. Last updated: 2022-08-02.

Intended use: Treatment of malignant mesothelioma

Source: https://www.ema.europa.eu/en/medicines/human/orphan-designations/eu-3-14-1409